EMA orphan designation EU/3/21/2452
Active substance: Hydrocortisone hydrogen succinate
Designated 2021-06-21. Orphan designation status: Withdrawn.
First published: 2022-05-11.

Intended use: Prevention of bronchopulmonary dysplasia

Source: https://www.ema.europa.eu/en/medicines/human/orphan-designations/eu-3-21-2452